EMA orphan designation EU/3/19/2169
Active substance: reldesemtiv
Designated 2019-06-28. Orphan designation status: Positive.
First published: 2019-10-02. Last updated: 2024-10-11.

Intended use: Treatment of spinal muscular atrophy

Source: https://www.ema.europa.eu/en/medicines/human/orphan-designations/eu-3-19-2169